EMA orphan designation EU/3/10/824
Active substance: N-[(2S)-2,3-dihydroxypropyl]-3-[(2-fluoro-4-iodophenyl)amino]isonicotinamide hydrochloride
Designated 2010-12-17. Orphan designation status: Withdrawn.
First published: 2011-01-20. Last updated: 2020-08-12.

Intended use: Treatment of acute myeloid leukaemia

Source: https://www.ema.europa.eu/en/medicines/human/orphan-designations/eu-3-10-824